EMA orphan designation EU/3/23/2785
Active substance: doruxapapogene ralaplasmid
Designated 2023-05-22. Orphan designation status: Positive.
First published: 2024-01-25.

Intended use: Treatment of recurrent respiratory papillomatosis

Source: https://www.ema.europa.eu/en/medicines/human/orphan-designations/eu-3-23-2785